EMA orphan designation EU/3/20/2337
Active substance: ribitol
Designated 2020-10-19. Orphan designation status: Positive.
First published: 2021-05-25. Last updated: 2023-02-27.

Intended use: Treatment of limb-girdle muscular dystrophy

Source: https://www.ema.europa.eu/en/medicines/human/orphan-designations/eu-3-20-2337